EMA orphan designation EU/3/08/539
Active substance: ammonium tetrathiomolybdate
Designated 2008-04-01. Orphan designation status: Positive.
First published: 2008-07-10. Last updated: 2008-07-10.

Intended use: Treatment of Wilson's disease

Source: https://www.ema.europa.eu/en/medicines/human/orphan-designations/eu-3-08-539